EMA orphan designation EU/3/18/1980
Active substance: Levosimendan
Designated 2018-02-22. Orphan designation status: Withdrawn.
First published: 2018-04-11. Last updated: 2021-03-19.

Intended use: Treatment of amyotrophic lateral sclerosis

Source: https://www.ema.europa.eu/en/medicines/human/orphan-designations/eu-3-18-1980